EMA orphan designation EU/3/24/2902
Active substance: alrefimotide acetate; riletamotide acetate; tapderimotide acetate
Designated 2024-03-21. Orphan designation status: Withdrawn.
First published: 2024-07-23. Last updated: 2025-04-09.

Intended use: Treatment of mesothelioma

Source: https://www.ema.europa.eu/en/medicines/human/orphan-designations/eu-3-24-2902